EMA orphan designation EU/3/11/863
Active substance: recombinant fusion protein linking human coagulation factor VIIa with human albumin
Designated 2011-05-13. Orphan designation status: Positive.
First published: 2011-05-31. Last updated: 2018-03-28.

Intended use: Treatment of haemophilia B

Source: https://www.ema.europa.eu/en/medicines/human/orphan-designations/eu-3-11-863